EMA orphan designation EU/3/25/3115
Active substance: gildeuretinol, gildeuretinol acetate
Designated 2025-08-22. Orphan designation status: Positive.
First published: 2026-04-10.

Intended use: Treatment of non-syndromic inherited retinal dystrophies due to defects in the ABCA4 gene

Source: https://www.ema.europa.eu/en/medicines/human/orphan-designations/eu-3-25-3115